EMA orphan designation EU/3/04/191
Active substance: Ethanol
Designated 2004-03-09. Orphan designation status: Withdrawn.
First published: 2005-04-28. Last updated: 2016-01-18.

Intended use: Treatment of congenital venous malformations

Source: https://www.ema.europa.eu/en/medicines/human/orphan-designations/eu-3-04-191